EMA orphan designation EU/3/07/481
Active substance: R-salbutamol sulfate
Designated 2007-09-14. Orphan designation status: Positive.
First published: 2008-07-02. Last updated: 2008-07-02.

Intended use: Treatment of cutaneus forms of lupus erythromatosus

Source: https://www.ema.europa.eu/en/medicines/human/orphan-designations/eu-3-07-481